EMA orphan designation EU/3/16/1721
Active substance: L-Pyr-L-Glu-L-Gln-L-Leu-L-Glu-L-Arg-L-Ala-L-Leu-L-Asn-L-Ser-L-Ser (cibinetide)
Designated 2016-08-29. Orphan designation status: Positive.
First published: 2016-10-03. Last updated: 2016-10-03.

Intended use: Prevention of graft loss in pancreatic islet transplantation

Source: https://www.ema.europa.eu/en/medicines/human/orphan-designations/eu-3-16-1721